EMA orphan designation EU/3/08/585
Active substance: daunorubicin
Designated 2008-12-03. Orphan designation status: Positive.
First published: 2009-10-30. Last updated: 2009-10-30.

Intended use: Treatment of acute myeloid leukaemia

Source: https://www.ema.europa.eu/en/medicines/human/orphan-designations/eu-3-08-585